EMA orphan designation EU/3/19/2216: Pemazyre
Active substance: pemigatinib
Designated 2019-10-17. Orphan designation status: Positive. EMA product number: EMEA/H/C/005266.
First published: 2020-01-23. Last updated: 2021-02-01.

Intended use: Treatment of myeloid/lymphoid neoplasms with eosinophilia and rearrangement of PDGFRA, PDGFRB, or FGFR1, or with PCM1-JAK

Source: https://www.ema.europa.eu/en/medicines/human/orphan-designations/eu-3-19-2216